EMA orphan designation EU/3/08/550
Active substance: nimotuzumab
Designated 2008-06-03. Orphan designation status: Positive.
First published: 2008-08-18. Last updated: 2018-10-12.

Intended use: Treatment of pancreatic cancer

Source: https://www.ema.europa.eu/en/medicines/human/orphan-designations/eu-3-08-550